EMA orphan designation EU/3/14/1369
Active substance: siponimod
Designated 2014-11-19. Orphan designation status: Withdrawn.
First published: 2015-01-16. Last updated: 2018-03-12.

Intended use: Treatment of dermatomyositis

Source: https://www.ema.europa.eu/en/medicines/human/orphan-designations/eu-3-14-1369